EMA orphan designation EU/3/23/2822
Active substance: Lithium carbonate
Designated 2023-08-16. Orphan designation status: Positive.
First published: 2024-01-25.

Intended use: Treatment of Kleine Levin syndrome

Source: https://www.ema.europa.eu/en/medicines/human/orphan-designations/eu-3-23-2822